EMA orphan designation EU/3/25/3178
Active substance: navtemadlin
Designated 2026-01-09. Orphan designation status: Positive.
First published: 2026-03-25.

Intended use: Treatment of myelofibrosis

Source: https://www.ema.europa.eu/en/medicines/human/orphan-designations/eu-3-25-3178